EMA orphan designation EU/3/25/3148
Active substance: 2-(3-Chlorophenyl)-N-[6-(trifluoromethyl)-1,3-benzothiazol-2-yl]acetamide
Designated 2025-11-21. Orphan designation status: Positive.
First published: 2026-04-10.

Intended use: Treatment of amyotrophic lateral sclerosis

Source: https://www.ema.europa.eu/en/medicines/human/orphan-designations/eu-3-25-3148